EMA orphan designation EU/3/07/464
Active substance: panobinostat lactate
Designated 2007-08-02. Orphan designation status: Withdrawn.
First published: 2008-01-22. Last updated: 2015-02-11.

Intended use: Treatment of cutaneous T-cell lymphoma

Source: https://www.ema.europa.eu/en/medicines/human/orphan-designations/eu-3-07-464